EMA orphan designation EU/3/06/361
Active substance: Glutathione
Designated 2006-04-11. Orphan designation status: Withdrawn.
First published: 2009-05-15. Last updated: 2014-10-16.

Intended use: Treatment of cystic fibrosis

Source: https://www.ema.europa.eu/en/medicines/human/orphan-designations/eu-3-06-361